EMA orphan designation EU/3/15/1509: Zolgensma; Itvisma
Active substance: adeno-associated viral vector serotype 9 containing the human SMN gene (onasemnogene abeparvovec)
Designated 2015-06-19. Orphan designation status: Positive. EMA product number: EMEA/H/C/004750; EMEA/H/C/006498.
First published: 2015-07-27. Last updated: 2022-07-25.

Intended use: Treatment of spinal muscular atrophy

Source: https://www.ema.europa.eu/en/medicines/human/orphan-designations/eu-3-15-1509